EMA orphan designation EU/3/04/206
Active substance: muramyl tripeptide phosphatidyl ethanolamine
Designated 2004-06-21. Orphan designation status: Expired.
First published: 2009-09-23. Last updated: 2019-07-11.

Intended use: Treatment of osteosarcoma

Source: https://www.ema.europa.eu/en/medicines/human/orphan-designations/eu-3-04-206